EMA orphan designation EU/3/14/1366
Active substance: recombinant human pentraxin-2
Designated 2014-11-19. Orphan designation status: Positive.
First published: 2015-01-15. Last updated: 2020-06-08.

Intended use: Treatment of primary myelofibrosis

Source: https://www.ema.europa.eu/en/medicines/human/orphan-designations/eu-3-14-1366